EMA orphan designation EU/3/02/126
Active substance: recombinant inhibitor of human plasma kallikrein
Designated 2002-12-18. Orphan designation status: Withdrawn.
First published: 2009-09-23. Last updated: 2014-10-16.

Intended use: Treatment of angioedema

Source: https://www.ema.europa.eu/en/medicines/human/orphan-designations/eu-3-02-126